EMA orphan designation EU/3/25/3107
Active substance: allogeneic cultured postnatal thymus-derived tissue
Designated 2025-08-22. Orphan designation status: Positive.
First published: 2026-04-10.

Intended use: Treatment of congenital athymia

Source: https://www.ema.europa.eu/en/medicines/human/orphan-designations/eu-3-25-3107